EMA orphan designation EU/3/14/1371
Active substance: Humanised IgG1 monoclonal antibody against human eotaxin-2
Designated 2014-11-19. Orphan designation status: Positive.
First published: 2015-01-16. Last updated: 2016-08-15.

Intended use: Treatment of systemic sclerosis

Source: https://www.ema.europa.eu/en/medicines/human/orphan-designations/eu-3-14-1371